EMA orphan designation EU/3/16/1718
Active substance: cannabidiol
Designated 2016-08-29. Orphan designation status: Positive.
First published: 2016-10-10. Last updated: 2016-10-10.

Intended use: Treatment of graft-versus-host disease

Source: https://www.ema.europa.eu/en/medicines/human/orphan-designations/eu-3-16-1718